EMA orphan designation EU/3/26/3225
Active substance: aganirsen
Designated 2026-04-20. Orphan designation status: Positive.
First published: 2026-04-22.

Intended use: Prevention of corneal graft rejection

Source: https://www.ema.europa.eu/en/medicines/human/orphan-designations/eu-3-26-3225